EMA orphan designation EU/3/23/2873
Active substance: 3,3-Dimethyl-N-(6-methyl-5-{[2-(1-methyl-1H-pyrazol-4-yl)pyridine-4-yl]oxy}pyridine-2-yl)-2-oxopyrrolidine-1-carboxamide hydrochloride hydrate
Designated 2023-12-13. Orphan designation status: Positive.
First published: 2024-02-01. Last updated: 2024-04-11.

Intended use: Treatment of tenosynovial giant cell tumour, localised and diffuse type

Source: https://www.ema.europa.eu/en/medicines/human/orphan-designations/eu-3-23-2873